EMA orphan designation EU/3/23/2828: Wainzua
Active substance: eplontersen
Designated 2023-10-13. Orphan designation status: Withdrawn. EMA product number: EMEA/H/C/006295.
First published: 2024-01-24. Last updated: 2024-12-03.

Intended use: Treatment of transthyretin-mediated amyloidosis

Source: https://www.ema.europa.eu/en/medicines/human/orphan-designations/eu-3-23-2828